EMA orphan designation EU/3/15/1454
Active substance: 6-ethoxy-7-methoxy-2-(2-methylsulfanylphenyl)-3,1-benzoxazin-4-one
Designated 2015-03-19. Orphan designation status: Positive.
First published: 2015-05-11. Last updated: 2015-05-11.

Intended use: Treatment of Netherton syndrome

Source: https://www.ema.europa.eu/en/medicines/human/orphan-designations/eu-3-15-1454